EMA orphan designation EU/3/24/2908
Active substance: 4-(4-Methyl-piperazin-1-yl)-N-{6-[2-(4-trifluoromethyl-benzyloxy)-ethoxy]-1H-indazol-3-yl}-benzamide hemioxalate
Designated 2024-03-21. Orphan designation status: Positive.
First published: 2024-07-23.

Intended use: Treatment of acute myeloid leukaemia

Source: https://www.ema.europa.eu/en/medicines/human/orphan-designations/eu-3-24-2908